EMA orphan designation EU/3/22/2686
Active substance: Gold
Designated 2022-08-10. Orphan designation status: Positive.
First published: 2022-12-15.

Intended use: Treatment of amyotrophic lateral sclerosis

Source: https://www.ema.europa.eu/en/medicines/human/orphan-designations/eu-3-22-2686